EMA orphan designation EU/3/20/2263
Active substance: Losmapimod
Designated 2020-03-24. Orphan designation status: Withdrawn.
First published: 2020-08-12. Last updated: 2025-01-15.

Intended use: treatment of facioscapulohumeral muscular dystrophy

Source: https://www.ema.europa.eu/en/medicines/human/orphan-designations/eu-3-20-2263